EMA orphan designation EU/3/15/1432
Active substance: 2'-O-methyl phosphorothioate RNA oligonucleotide, 5'-m5CUGm5CUGm5CUGm5CUGm5CUGm5CUGm5CUG-3'
Designated 2015-02-12. Orphan designation status: Positive.
First published: 2015-04-08. Last updated: 2020-06-23.

Intended use: Treatment of Huntington’s disease

Source: https://www.ema.europa.eu/en/medicines/human/orphan-designations/eu-3-15-1432